EMA orphan designation EU/3/22/2592
Active substance: adeno-associated virus serotype HSC 15 expressing human iduronate 2-sulfatase (Bevufenogene nofeparvovec)
Designated 2022-03-16. Orphan designation status: Withdrawn.
First published: 2022-06-20. Last updated: 2023-08-08.

Intended use: Treatment of mucopolysaccharidosis type II (Hunter Syndrome)

Source: https://www.ema.europa.eu/en/medicines/human/orphan-designations/eu-3-22-2592